EMA orphan designation EU/3/15/1444
Active substance: Mazindol
Designated 2015-02-12. Orphan designation status: Withdrawn.
First published: 2015-04-09. Last updated: 2018-05-29.

Intended use: Treatment of narcolepsy

Source: https://www.ema.europa.eu/en/medicines/human/orphan-designations/eu-3-15-1444